EMA orphan designation EU/3/11/929
Active substance: adeno-associated viral vector serotype 8 containing the human AIPL1 gene
Designated 2011-12-09. Orphan designation status: Withdrawn.
First published: 2011-12-20. Last updated: 2015-03-06.

Intended use: Treatment of Leber's congenital amaurosis type 4

Source: https://www.ema.europa.eu/en/medicines/human/orphan-designations/eu-3-11-929